EMA orphan designation EU/3/11/868
Active substance: lenalidomide
Designated 2011-05-13. Orphan designation status: Withdrawn.
First published: 2011-05-30. Last updated: 2020-01-13.

Intended use: Treatment of diffuse large B-cell lymphoma

Source: https://www.ema.europa.eu/en/medicines/human/orphan-designations/eu-3-11-868